EMA orphan designation EU/3/24/2977
Active substance: glecirasib
Designated 2024-11-10. Orphan designation status: Positive.
First published: 2024-11-19.

Intended use: Treatment of pancreatic cancer

Source: https://www.ema.europa.eu/en/medicines/human/orphan-designations/eu-3-24-2977